EMA orphan designation EU/3/18/2126
Active substance: human glucagon-like peptide-2 analogue linked to a human immunoglobulin Fc fragment
Designated 2019-01-11. Orphan designation status: Positive.
First published: 2019-03-27.

Intended use: Treatment of short bowel syndrome

Source: https://www.ema.europa.eu/en/medicines/human/orphan-designations/eu-3-18-2126